EMA orphan designation EU/3/24/2913
Active substance: acetylleucine
Designated 2024-04-16. Orphan designation status: Positive.
First published: 2024-07-26.

Intended use: Treatment of ataxia-oculomotor apraxia

Source: https://www.ema.europa.eu/en/medicines/human/orphan-designations/eu-3-24-2913